EMA orphan designation EU/3/07/473
Active substance: aviptadil
Designated 2007-09-14. Orphan designation status: Positive.
First published: 2008-01-22. Last updated: 2008-01-22.

Intended use: Treatment of sarcoidosis

Source: https://www.ema.europa.eu/en/medicines/human/orphan-designations/eu-3-07-473